EMA orphan designation EU/3/15/1534: Daybu
Active substance: Glycyl-L-2-methylprolyl-L-glutamic acid; trofinetide
Designated 2015-08-10. Orphan designation status: Positive. EMA product number: EMEA/H/C/006482.
First published: 2015-10-02. Last updated: 2024-10-22.

Intended use: Treatment of Rett syndrome

Source: https://www.ema.europa.eu/en/medicines/human/orphan-designations/eu-3-15-1534